EMA orphan designation EU/3/12/979
Active substance: sodium thiosulfate
Designated 2012-04-02. Orphan designation status: Positive.
First published: 2012-04-30. Last updated: 2012-04-30.

Intended use: Treatment of calciphylaxis

Source: https://www.ema.europa.eu/en/medicines/human/orphan-designations/eu-3-12-979